EMA orphan designation EU/3/14/1408
Active substance: 5,5'-(4-(trifluromethyl)benzylazanediyl)bis(methylene)diquinolin-8-ol
Designated 2014-12-16. Orphan designation status: Positive.
First published: 2015-02-27. Last updated: 2015-02-27.

Intended use: Treatment of glioma

Source: https://www.ema.europa.eu/en/medicines/human/orphan-designations/eu-3-14-1408